EMA orphan designation EU/3/15/1561
Active substance: ataluren
Designated 2015-10-09. Orphan designation status: Withdrawn.
First published: 2015-11-18. Last updated: 2026-05-18.

Intended use: Treatment of aniridia

Source: https://www.ema.europa.eu/en/medicines/human/orphan-designations/eu-3-15-1561